EMA orphan designation EU/3/16/1642
Active substance: autologous dermal fibroblasts genetically modified ex vivo with a lentiviral vector containing the human COL7A1 gene
Designated 2016-04-28. Orphan designation status: Positive.
First published: 2016-05-30. Last updated: 2016-05-30.

Intended use: Treatment of epidermolysis bullosa

Source: https://www.ema.europa.eu/en/medicines/human/orphan-designations/eu-3-16-1642